EMA orphan designation EU/3/25/3037
Active substance: ixodes ricinus contact phase inhibitor
Designated 2025-03-25. Orphan designation status: Positive.
First published: 2025-04-04.

Intended use: Treatment of non-traumatic spontaneous intracerebral haemorrhage

Source: https://www.ema.europa.eu/en/medicines/human/orphan-designations/eu-3-25-3037